EMA orphan designation EU/3/19/2210: Casgevy
Active substance: autologous CD34+ haematopoietic stem cells with a CRISPR-edited erythroid enhancer region of the BCL11A gene
Designated 2019-10-17. Orphan designation status: Positive. EMA product number: EMEA/H/C/005763.
First published: 2020-01-22. Last updated: 2022-01-25.

Intended use: Treatment of beta thalassaemia intermedia and major

Source: https://www.ema.europa.eu/en/medicines/human/orphan-designations/eu-3-19-2210